EMA orphan designation EU/3/16/1672
Active substance: 3-(5-Amino-2-methyl-4-oxoquinazolin-3(4H)-yl)piperidine-2,6-dione hydrochloride
Designated 2016-06-27. Orphan designation status: Withdrawn.
First published: 2016-07-26. Last updated: 2019-01-23.

Intended use: Treatment of diffuse large B-cell lymphoma

Source: https://www.ema.europa.eu/en/medicines/human/orphan-designations/eu-3-16-1672